EMA orphan designation EU/3/16/1733
Active substance: temozolomide
Designated 2016-08-29. Orphan designation status: Positive.
First published: 2016-10-03. Last updated: 2016-10-03.

Intended use: Treatment of glioma

Source: https://www.ema.europa.eu/en/medicines/human/orphan-designations/eu-3-16-1733